EMA orphan designation EU/3/10/734: Ravicti
Active substance: Glyceryl tri-(4-phenylbutyrate)
Designated 2010-06-10. Orphan designation status: Positive. EMA product number: EMEA/H/C/003822.
First published: 2010-06-24. Last updated: 2022-11-18.

Intended use: Treatment of ornithine carbamoyltransferase deficiency

Source: https://www.ema.europa.eu/en/medicines/human/orphan-designations/eu-3-10-734